EMA orphan designation EU/3/24/2948
Active substance: adeno-associated virus vector serotype 8 containing the human TYMP gene (CpG-depleted)
Designated 2024-07-25. Orphan designation status: Positive.
First published: 2024-09-13.

Intended use: Treatment of mitochondrial neurogastrointestinal encephalomyopathy (MNGIE)

Source: https://www.ema.europa.eu/en/medicines/human/orphan-designations/eu-3-24-2948